EMA orphan designation EU/3/12/988
Active substance: Halofuginone hydrobromide
Designated 2012-04-26. Orphan designation status: Withdrawn.
First published: 2012-06-18. Last updated: 2019-02-21.

Intended use: Treatment of Duchenne muscular dystrophy

Source: https://www.ema.europa.eu/en/medicines/human/orphan-designations/eu-3-12-988